EMA orphan designation EU/3/06/363
Active substance: 4-[131I] Iodo-L-phenylalanine
Designated 2006-04-11. Orphan designation status: Positive.
First published: 2009-04-24. Last updated: 2022-12-19.

Intended use: Treatment of glioma

Source: https://www.ema.europa.eu/en/medicines/human/orphan-designations/eu-3-06-363